EMA orphan designation EU/3/06/382
Active substance: pazopanib hydrochloride
Designated 2006-06-29. Orphan designation status: Withdrawn.
First published: 2009-02-20. Last updated: 2011-06-01.

Intended use: Treatment of renal-cell carcinoma

Source: https://www.ema.europa.eu/en/medicines/human/orphan-designations/eu-3-06-382